EMA orphan designation EU/3/02/127
Active substance: cholic acid
Designated 2002-12-18. Orphan designation status: Positive.
First published: 2008-08-18. Last updated: 2023-09-25.

Intended use: Treatment of inborn errors in primary bile acid synthesis

Source: https://www.ema.europa.eu/en/medicines/human/orphan-designations/eu-3-02-127